EMA orphan designation EU/3/23/2819
Active substance: virus-like particle containing Cas9/gRNA ribonucleoprotein targeting the human HTT gene
Designated 2023-08-16. Orphan designation status: Positive.
First published: 2024-02-02.

Intended use: Treatment of Huntington’s disease

Source: https://www.ema.europa.eu/en/medicines/human/orphan-designations/eu-3-23-2819